EMA orphan designation EU/3/23/2889
Active substance: mitapivat sulfate
Designated 2024-01-12. Orphan designation status: Positive.
First published: 2024-04-11.

Intended use: Treatment of thalassaemia intermedia and major

Source: https://www.ema.europa.eu/en/medicines/human/orphan-designations/eu-3-23-2889